EMA orphan designation EU/3/21/2463
Active substance: Itolizumab
Designated 2021-07-19. Orphan designation status: Positive.
First published: 2021-11-12.

Intended use: Treatment of graft-versus-host disease

Source: https://www.ema.europa.eu/en/medicines/human/orphan-designations/eu-3-21-2463